EMA orphan designation EU/3/24/2910
Active substance: annamycin
Designated 2024-04-16. Orphan designation status: Positive.
First published: 2024-07-26.

Intended use: Treatment of acute myeloid leukaemia

Source: https://www.ema.europa.eu/en/medicines/human/orphan-designations/eu-3-24-2910